EMA orphan designation EU/3/13/1214: Livmarli
Active substance: (4R,5R)-1-[[4-[[4-[3,3-dibutyl-7-(dimethylamino)-2,3,4,5- tetrahydro-4-hydroxy-1,1-dioxido-1-benzothiepin-5-yl]phenoxy]methyl]phenyl]methyl]-4-aza-1-azoniabicyclo[2.2.2]octane chloride (maralixibat chloride)
Designated 2013-12-18. Orphan designation status: Positive. EMA product number: EMEA/H/C/005857.
First published: 2014-01-22. Last updated: 2022-12-14.

Intended use: Treatment of Alagille syndrome

Source: https://www.ema.europa.eu/en/medicines/human/orphan-designations/eu-3-13-1214